EMA orphan designation EU/3/14/1368: Sohonos
Active substance: palovarotene
Designated 2014-11-19. Orphan designation status: Withdrawn. EMA product number: EMEA/H/C/004867.
First published: 2015-01-15. Last updated: 2019-07-18.

Intended use: Treatment of fibrodysplasia ossificans progressiva

Source: https://www.ema.europa.eu/en/medicines/human/orphan-designations/eu-3-14-1368